EMA orphan designation EU/3/23/2795
Active substance: Efzofitimod
Designated 2023-06-20. Orphan designation status: Positive.
First published: 2024-02-02.

Intended use: Treatment of systemic sclerosis

Source: https://www.ema.europa.eu/en/medicines/human/orphan-designations/eu-3-23-2795